EMA orphan designation EU/3/14/1346
Active substance: oxalobacter formigenes strain HC-1
Designated 2014-10-15. Orphan designation status: Positive.
First published: 2014-11-12. Last updated: 2021-02-02.

Intended use: Treatment of short bowel syndrome

Source: https://www.ema.europa.eu/en/medicines/human/orphan-designations/eu-3-14-1346